EMA orphan designation EU/3/07/485
Active substance: alvocidib
Designated 2007-10-23. Orphan designation status: Positive.
First published: 2008-08-18. Last updated: 2022-03-07.

Intended use: Treatment of chronic lymphocytic leukaemia

Source: https://www.ema.europa.eu/en/medicines/human/orphan-designations/eu-3-07-485